EMA orphan designation EU/3/09/640
Active substance: pegylated recombinant human factor IX (nonacog beta pegol)
Designated 2009-05-15. Orphan designation status: Expired.
First published: 2009-06-09. Last updated: 2017-08-03.

Intended use: Treatment of haemophilia B

Source: https://www.ema.europa.eu/en/medicines/human/orphan-designations/eu-3-09-640